EMA orphan designation EU/3/10/828
Active substance: silibinin-C-2',3-dihydrogensuccinate, disodium salt
Designated 2010-12-17. Orphan designation status: Positive.
First published: 2011-01-20. Last updated: 2011-01-20.

Intended use: Prevention of recurrent hepatitis C in liver transplant recipients

Source: https://www.ema.europa.eu/en/medicines/human/orphan-designations/eu-3-10-828